EMA orphan designation EU/3/09/682
Active substance: anti-EphA2 monoclonal antibody conjugated to maleimidocaproyl monomethylauristatin phenylalanine
Designated 2009-10-28. Orphan designation status: Withdrawn.
First published: 2009-11-18. Last updated: 2010-11-19.

Intended use: Treatment of ovarian cancer

Source: https://www.ema.europa.eu/en/medicines/human/orphan-designations/eu-3-09-682